EMA orphan designation EU/3/26/3227
Active substance: daraxonrasib
Designated 2026-04-20. Orphan designation status: Positive.
First published: 2026-04-22.

Intended use: Treatment of pancreatic cancer

Source: https://www.ema.europa.eu/en/medicines/human/orphan-designations/eu-3-26-3227